EMA orphan designation EU/3/02/106
Active substance: antisense NF-kBp65 oligonucleotide
Designated 2002-07-30. Orphan designation status: Withdrawn.
First published: 2003-01-23. Last updated: 2026-05-26.

Intended use: Treatment of active ulcerative colitis

Source: https://www.ema.europa.eu/en/medicines/human/orphan-designations/eu-3-02-106